EMA orphan designation EU/3/17/1922
Active substance: Entospletinib
Designated 2017-10-16. Orphan designation status: Withdrawn.
First published: 2018-01-08. Last updated: 2025-06-10.

Intended use: Treatment of acute myeloid leukaemia

Source: https://www.ema.europa.eu/en/medicines/human/orphan-designations/eu-3-17-1922